EMA orphan designation EU/3/15/1516
Active substance: modified adenovirus serotype 5/35 containing a CMV promoter-driven transgene cassette with the human transgenes for a membrane-bound CD40 ligand and full length 4-1BBL
Designated 2015-07-28. Orphan designation status: Positive.
First published: 2015-08-18. Last updated: 2020-06-11.

Intended use: Treatment of pancreatic cancer

Source: https://www.ema.europa.eu/en/medicines/human/orphan-designations/eu-3-15-1516